EMA orphan designation EU/3/13/1172
Active substance: Idelalisib
Designated 2013-08-05. Orphan designation status: Withdrawn.
First published: 2013-09-12. Last updated: 2013-11-18.

Intended use: Treatment of nodal marginal-zone lymphoma

Source: https://www.ema.europa.eu/en/medicines/human/orphan-designations/eu-3-13-1172